EMA orphan designation EU/3/12/1040: Bylvay
Designated 2012-08-09. Orphan designation status: Withdrawn. EMA product number: EMEA/H/C/004691.
First published: 2012-09-26. Last updated: 2026-05-26.

Intended use: Treatment of Alagille syndrome

Source: https://www.ema.europa.eu/en/medicines/human/orphan-designations/eu-3-12-1040